EMA orphan designation EU/3/11/879
Active substance: chimeric monoclonal antibody against GD2 (dinutuximab)
Designated 2011-06-21. Orphan designation status: Withdrawn.
First published: 2011-07-12. Last updated: 2019-02-21.

Intended use: Treatment of neuroblastoma

Source: https://www.ema.europa.eu/en/medicines/human/orphan-designations/eu-3-11-879